EMA orphan designation EU/3/08/601
Active substance: Milatuzumab
Designated 2009-01-19. Orphan designation status: Withdrawn.
First published: 2009-06-29. Last updated: 2020-02-18.

Intended use: Treatment of multiple myeloma

Source: https://www.ema.europa.eu/en/medicines/human/orphan-designations/eu-3-08-601